EMA orphan designation EU/3/10/773
Active substance: allogeneic T cells encoding an exogenous TK gene
Designated 2010-09-20. Orphan designation status: Positive.
First published: 2010-10-18. Last updated: 2010-10-18.

Intended use: Treatment of acute myeloid leukaemia

Source: https://www.ema.europa.eu/en/medicines/human/orphan-designations/eu-3-10-773